EMA orphan designation EU/3/07/475
Active substance: alginate oligosaccharide (G-block) fragment
Designated 2007-09-14. Orphan designation status: Positive.
First published: 2009-09-17. Last updated: 2009-09-17.

Intended use: Treatment of cystic fibrosis

Source: https://www.ema.europa.eu/en/medicines/human/orphan-designations/eu-3-07-475